EMA orphan designation EU/3/24/2935
Active substance: (R)-(3-(2'-cyclopropyl-3-(hydroxymethyl)-[1,1'-biphenyl]-4-yl) pyrrolidin-1-yl)(5-fluoropyridin-2-yl)methanone
Designated 2024-06-28. Orphan designation status: Positive.
First published: 2024-08-22.

Intended use: Treatment of pachyonychia congenita

Source: https://www.ema.europa.eu/en/medicines/human/orphan-designations/eu-3-24-2935